EMA orphan designation EU/3/10/728
Active substance: Davunetide
Designated 2010-03-23. Orphan designation status: Withdrawn.
First published: 2010-03-26. Last updated: 2013-06-25.

Intended use: Treatment of progressive supranuclear palsy

Source: https://www.ema.europa.eu/en/medicines/human/orphan-designations/eu-3-10-728